EMA orphan designation EU/3/17/1958
Active substance: allogeneic umbilical cord blood CD34+ cells cultured ex vivo with Notch ligand Delta1 (dilanubicel)
Designated 2018-01-17. Orphan designation status: Withdrawn.
First published: 2018-03-14. Last updated: 2021-03-22.

Intended use: Treatment in haematopoietic stem cell transplantation

Source: https://www.ema.europa.eu/en/medicines/human/orphan-designations/eu-3-17-1958